EMA orphan designation EU/3/08/574
Active substance: autologous urothelial and smooth muscle cells
Designated 2008-11-08. Orphan designation status: Withdrawn.
First published: 2009-04-24. Last updated: 2012-05-24.

Intended use: Treatment of spinal cord injury

Source: https://www.ema.europa.eu/en/medicines/human/orphan-designations/eu-3-08-574